EMA orphan designation EU/3/16/1742
Active substance: acebutolol hydrochloride
Designated 2016-10-14. Orphan designation status: Positive.
First published: 2016-11-15. Last updated: 2019-03-18.

Intended use: Treatment of Smith-Magenis syndrome

Source: https://www.ema.europa.eu/en/medicines/human/orphan-designations/eu-3-16-1742